EMA orphan designation EU/3/14/1292
Active substance: carboxy pyrrolidine hexanoyl pyrrolidine carboxylate (miridesap)
Designated 2014-07-29. Orphan designation status: Withdrawn.
First published: 2014-09-01. Last updated: 2019-07-09.

Intended use: Treatment of AL amyloidosis

Source: https://www.ema.europa.eu/en/medicines/human/orphan-designations/eu-3-14-1292